EMA orphan designation EU/3/22/2585
Active substance: dersimelagon
Designated 2022-03-16. Orphan designation status: Positive.
First published: 2022-06-16. Last updated: 2023-12-07.

Intended use: Treatment of erythropoietic protoporphyria

Source: https://www.ema.europa.eu/en/medicines/human/orphan-designations/eu-3-22-2585